EMA orphan designation EU/3/07/477
Active substance: 4-Amino-1-[5-O-[(2R,4S)-2-oxido-4-(4-pyridinyl)-1,3,2-dioxaphosphorinan-2-yl]-ß-D-arabinofuranosyl]-2(1H)-pyrimidinone
Designated 2007-09-14. Orphan designation status: Positive.
First published: 2008-01-22. Last updated: 2008-01-22.

Intended use: Treatment of hepatocellular carcinoma

Source: https://www.ema.europa.eu/en/medicines/human/orphan-designations/eu-3-07-477